EMA orphan designation EU/3/14/1365
Active substance: recombinant human pentraxin-2
Designated 2014-11-19. Orphan designation status: Positive.
First published: 2015-01-15. Last updated: 2020-06-08.

Intended use: Treatment of post-polycythaemia vera myelofibrosis

Source: https://www.ema.europa.eu/en/medicines/human/orphan-designations/eu-3-14-1365